EMA orphan designation EU/3/16/1818
Active substance: human hepatoma cell line HepaRG in bioartificial liver
Designated 2017-01-12. Orphan designation status: Positive.
First published: 2017-07-14. Last updated: 2017-07-14.

Intended use: Treatment of acute liver failure

Source: https://www.ema.europa.eu/en/medicines/human/orphan-designations/eu-3-16-1818